EMA orphan designation EU/3/16/1731: Givlaari
Active substance: synthetic double-stranded siRNA oligonucleotide directed against delta-aminolevulinic acid synthase 1 mRNA, covalently linked to a ligand containing three N-acetylgalactosamine residues (givosiran)
Designated 2016-08-29. Orphan designation status: Positive. EMA product number: EMEA/H/C/004775.
First published: 2016-10-11. Last updated: 2020-03-09.

Intended use: Treatment of acute hepatic porphyria

Source: https://www.ema.europa.eu/en/medicines/human/orphan-designations/eu-3-16-1731